EMA orphan designation EU/3/09/709
Active substance: recombinant fusion protein consisting of the extracellular portion of CD95 fused to the Fc part of a human IgG1 molecule
Designated 2010-01-28. Orphan designation status: Withdrawn.
First published: 2010-02-25. Last updated: 2023-09-15.

Intended use: Treatment of glioma

Source: https://www.ema.europa.eu/en/medicines/human/orphan-designations/eu-3-09-709